EMA orphan designation EU/3/11/859
Active substance: [N-((2S,3R,3aS,3'R,4a'R,6S,6a'R,6b'S,7aR,12a'S,12b'S,Z)-3,6,11',12b'-tetramethyl-2',3a,3',4,4',4a',5,5',6,6',6a',6b',7,7a,7',8',10',12',12a',12b'-icosahydro-1'H,3H-spiro[furo[3,2-b]pyridine-2,9'-naphtho[2,1-a]azulene]-3'-yl)methanesulfonamide hydrochloride]
Designated 2011-05-13. Orphan designation status: Withdrawn.
First published: 2011-05-27. Last updated: 2013-07-31.

Intended use: Treatment of chondrosarcoma

Source: https://www.ema.europa.eu/en/medicines/human/orphan-designations/eu-3-11-859